EMA orphan designation EU/3/22/2622
Active substance: losartan
Designated 2022-06-21. Orphan designation status: Positive.
First published: 2022-09-15.

Intended use: Treatment of osteogenesis imperfecta

Source: https://www.ema.europa.eu/en/medicines/human/orphan-designations/eu-3-22-2622